EMA orphan designation EU/3/26/3221
Active substance: oremepermin alfa
Designated 2026-04-20. Orphan designation status: Positive.
First published: 2026-04-22.

Intended use: Treatment of spinal cord injury

Source: https://www.ema.europa.eu/en/medicines/human/orphan-designations/eu-3-26-3221